EMA orphan designation EU/3/12/1008
Active substance: human erythrocytes encapsulating inositol hexaphosphate
Designated 2012-07-04. Orphan designation status: Positive.
First published: 2012-07-24. Last updated: 2012-07-24.

Intended use: Treatment of sickle cell disease

Source: https://www.ema.europa.eu/en/medicines/human/orphan-designations/eu-3-12-1008